EMA orphan designation EU/3/08/565
Active substance: drotrecogin alfa (activated)
Designated 2008-09-22. Orphan designation status: Withdrawn.
First published: 2009-02-24. Last updated: 2021-09-21.

Intended use: Treatment of acute respiratory distress syndrome (ARDS)

Source: https://www.ema.europa.eu/en/medicines/human/orphan-designations/eu-3-08-565